EMA orphan designation EU/3/07/465
Active substance: L-threo-3,4-dihydroxyphenylserine
Designated 2007-08-02. Orphan designation status: Withdrawn.
First published: 2009-09-21. Last updated: 2022-01-28.

Intended use: Treatment of orthostatic hypotension in patients with pure autonomic failure

Source: https://www.ema.europa.eu/en/medicines/human/orphan-designations/eu-3-07-465